EMA orphan designation EU/3/10/798
Active substance: synthetic double-stranded short interfering RNA oligonucleotide directed against proopiomelanocortin
Designated 2010-10-01. Orphan designation status: Positive.
First published: 2010-10-14. Last updated: 2013-02-28.

Intended use: Treatment of adrenocorticotropin-dependent Cushing's syndrome

Source: https://www.ema.europa.eu/en/medicines/human/orphan-designations/eu-3-10-798